EMA orphan designation EU/3/11/872
Active substance: sulfonated monophosphorylated mannose oligosaccharide
Designated 2011-06-21. Orphan designation status: Withdrawn.
First published: 2011-07-12. Last updated: 2023-06-09.

Intended use: Treatment of hepatocellular carcinoma

Source: https://www.ema.europa.eu/en/medicines/human/orphan-designations/eu-3-11-872